EMA orphan designation EU/3/26/3222
Active substance: 3-(4-((7-Cyano-1-methyl-2-((1-methyl-2-oxo-5-(trifluoromethyl)-1,2-dihydropyridin-3-yl)amino)-1H-imidazo[4,5-b]pyridin-6-yl)oxy)pyridin-2-yl)-1,1-dimethylurea
Designated 2026-04-20. Orphan designation status: Positive.
First published: 2026-04-22.

Intended use: Treatment of myelofibrosis

Source: https://www.ema.europa.eu/en/medicines/human/orphan-designations/eu-3-26-3222